EMA orphan designation EU/3/05/301
Active substance: chimeric monoclonal antibody to shiga toxin 1 and 2
Designated 2005-08-26. Orphan designation status: Positive.
First published: 2006-02-09. Last updated: 2019-04-12.

Intended use: Treatment of shiga-toxin-producing bacterial infection

Source: https://www.ema.europa.eu/en/medicines/human/orphan-designations/eu-3-05-301